EMA orphan designation EU/3/18/2072
Active substance: 6-(R)-methyl-5-O-(5-amino-5,6-dideoxy-alpha-L-talofuranosyl)-paromamine sulfate
Designated 2018-10-26. Orphan designation status: Positive.
First published: 2019-01-11. Last updated: 2022-05-24.

Intended use: Treatment of cystic fibrosis

Source: https://www.ema.europa.eu/en/medicines/human/orphan-designations/eu-3-18-2072